EMA orphan designation EU/3/24/2959
Active substance: gallium (68Ga) boclatixafortide
Designated 2024-08-21. Orphan designation status: Positive.
First published: 2024-09-19.

Intended use: Diagnosis of marginal zone lymphoma

Source: https://www.ema.europa.eu/en/medicines/human/orphan-designations/eu-3-24-2959